EMA orphan designation EU/3/15/1579: Yescarta
Active substance: autologous T cells transduced with retroviral vector encoding an anti-CD19 CD28/CD3-zeta chimeric antigen receptor (axicabtagene ciloleucel)
Designated 2015-11-11. Orphan designation status: Positive. EMA product number: EMEA/H/C/004480.
First published: 2016-01-06. Last updated: 2022-07-12.

Intended use: Treatment of follicular lymphoma

Source: https://www.ema.europa.eu/en/medicines/human/orphan-designations/eu-3-15-1579